EMA orphan designation EU/3/05/328: Istodax
Active substance: (E)-(1S,4S,10S,21R)-7-[(Z)-ethylidene]-4,21-diisopropyl-2-oxa-12,13-dithia-5,8,20,23- tetraazabicyclo[8.7.6]tricos-16-ene-3,6,9,19,22-pentone
Designated 2005-10-28. Orphan designation status: Positive. EMA product number: EMEA/H/C/002122.
First published: 2009-09-17. Last updated: 2018-10-12.

Intended use: Treatment of peripheral T-cell lymphoma (nodal, other extranodal and leukaemic/disseminated)

Source: https://www.ema.europa.eu/en/medicines/human/orphan-designations/eu-3-05-328